EMA orphan designation EU/3/15/1440
Active substance: allogeneic CD4+ and CD8+ T lymphocytes ex vivo incubated with synthetic peptides of the viral antigens of cytomegalovirus, adenovirus and Epstein-Barr virus
Designated 2015-03-19. Orphan designation status: Positive.
First published: 2015-05-19. Last updated: 2015-05-19.

Intended use: Treatment of Epstein-Barr virus infection following haematopoietic stem cell transplantation

Source: https://www.ema.europa.eu/en/medicines/human/orphan-designations/eu-3-15-1440